EMA orphan designation EU/3/15/1531
Active substance: sarizotan hydrochloride
Designated 2015-07-28. Orphan designation status: Positive.
First published: 2015-08-18. Last updated: 2015-08-18.

Intended use: Treatment of Rett syndrome

Source: https://www.ema.europa.eu/en/medicines/human/orphan-designations/eu-3-15-1531